EMA orphan designation EU/3/06/384
Active substance: Human telomerase reverse transcriptase peptide (611-626) (tertomotide)
Designated 2006-07-25. Orphan designation status: Withdrawn.
First published: 2009-09-21. Last updated: 2023-08-08.

Intended use: Treatment of pancreatic cancer

Source: https://www.ema.europa.eu/en/medicines/human/orphan-designations/eu-3-06-384